EMA orphan designation EU/3/11/870
Active substance: S-Nitrosoglutathione
Designated 2011-05-13. Orphan designation status: Positive.
First published: 2011-05-31. Last updated: 2019-05-13.

Intended use: Treatment of pre-eclampsia

Source: https://www.ema.europa.eu/en/medicines/human/orphan-designations/eu-3-11-870